EMA orphan designation EU/3/15/1602
Active substance: Live attenuated Listeria monocytogenes transfected with plasmids encoding the HPV-16E7 protein fused to a truncated fragment of the Lm protein listeriolysin O
Designated 2016-01-11. Orphan designation status: Withdrawn.
First published: 2016-02-05. Last updated: 2024-11-19.

Intended use: Treatment of anal cancer

Source: https://www.ema.europa.eu/en/medicines/human/orphan-designations/eu-3-15-1602